EMA orphan designation EU/3/14/1313
Active substance: (Z)-3-(3-(3,5-bis(trifluoromethyl)phenyl)-1H-1,2,4-triazol-1-yl)-N'-(pyrazin-2-yl)acrylohydrazide
Designated 2014-08-22. Orphan designation status: Positive.
First published: 2014-09-30. Last updated: 2026-05-11.

Intended use: Treatment of acute myeloid leukaemia

Source: https://www.ema.europa.eu/en/medicines/human/orphan-designations/eu-3-14-1313